EMA orphan designation EU/3/01/083: Cerepro
Active substance: adenovirus-mediated herpes-simplex-virus thymidine-kinase (HKSV-tk) gene
Designated 2002-02-06. Orphan designation status: Positive. EMA product number: EMEA/H/C/000694; EMEA/H/C/001103.
First published: 2003-01-06. Last updated: 2021-09-16.

Intended use: Treatment of high-grade glioma with subsequent use of ganciclovir sodium

Source: https://www.ema.europa.eu/en/medicines/human/orphan-designations/eu-3-01-083